EMA orphan designation EU/3/13/1199
Active substance: sorafenib tosilate
Designated 2013-11-13. Orphan designation status: Expired.
First published: 2013-12-02. Last updated: 2024-06-07.

Intended use: Treatment of follicular thyroid cancer

Source: https://www.ema.europa.eu/en/medicines/human/orphan-designations/eu-3-13-1199